EMA orphan designation EU/3/08/543
Active substance: sarsasapogenin
Designated 2008-05-08. Orphan designation status: Withdrawn.
First published: 2008-07-29. Last updated: 2012-09-06.

Intended use: Treatment of amyotrophic lateral sclerosis

Source: https://www.ema.europa.eu/en/medicines/human/orphan-designations/eu-3-08-543